EMA orphan designation EU/3/10/732
Active substance: Entinostat
Designated 2010-06-10. Orphan designation status: Positive.
First published: 2010-06-24. Last updated: 2023-09-13.

Intended use: Treatment of Hodgkin's lymphoma

Source: https://www.ema.europa.eu/en/medicines/human/orphan-designations/eu-3-10-732